EMA orphan designation EU/3/25/3166
Active substance: [4-(6-aminopyridazin-3-yl)piperidin-1-yl][5-(4-fluorophenoxy)-4-methoxypyridin-2-yl]methanone
Designated 2025-12-09. Orphan designation status: Positive.
First published: 2026-03-27.

Intended use: Treatment of focal segmental glomerulosclerosis

Source: https://www.ema.europa.eu/en/medicines/human/orphan-designations/eu-3-25-3166